EMA orphan designation EU/3/21/2521
Active substance: udonitrectag lysine
Designated 2021-11-12. Orphan designation status: Positive.
First published: 2022-06-08.

Intended use: Treatment in solid organ transplantation

Source: https://www.ema.europa.eu/en/medicines/human/orphan-designations/eu-3-21-2521